EMA orphan designation EU/3/01/030
Active substance: 8-cyclopentyl-1, 3-dipropylxanthine
Designated 2001-03-29. Orphan designation status: Withdrawn.
First published: 2009-05-15. Last updated: 2009-05-15.

Intended use: Treatment of cystic fibrosis

Source: https://www.ema.europa.eu/en/medicines/human/orphan-designations/eu-3-01-030